EMA orphan designation EU/3/14/1404
Active substance: 1-(2-isopropoxyethyl)-2-thioxo-1,2,3,5-tetrahydro-pyrrolo[3,2-d] pyrimidin-4-one (verdiperstat)
Designated 2014-12-16. Orphan designation status: Positive.
First published: 2015-02-27. Last updated: 2021-09-21.

Intended use: Treatment of multiple system atrophy

Source: https://www.ema.europa.eu/en/medicines/human/orphan-designations/eu-3-14-1404